EMA orphan designation EU/3/05/261: Retisert
Active substance: Fluocinolone acetonide
Designated 2005-03-07. Orphan designation status: Positive. EMA product number: EMEA/H/C/000787.
First published: 2007-05-30. Last updated: 2007-05-30.

Intended use: Treatment of non-infectious uveitis affecting the posterior segment of the eye

Source: https://www.ema.europa.eu/en/medicines/human/orphan-designations/eu-3-05-261